EMA orphan designation EU/3/13/1119
Active substance: lenvatinib
Designated 2013-04-26. Orphan designation status: Withdrawn.
First published: 2013-05-14. Last updated: 2018-10-22.

Intended use: Treatment of follicular thyroid cancer

Source: https://www.ema.europa.eu/en/medicines/human/orphan-designations/eu-3-13-1119